EMA orphan designation EU/3/18/2101: Imcivree
Active substance: setmelanotide
Designated 2018-11-19. Orphan designation status: Positive. EMA product number: EMEA/H/C/005089.
First published: 2019-02-25. Last updated: 2022-07-01.

Intended use: Treatment of leptin receptor deficiency

Source: https://www.ema.europa.eu/en/medicines/human/orphan-designations/eu-3-18-2101